EMA orphan designation EU/3/23/2841
Active substance: alpelisib
Designated 2023-10-13. Orphan designation status: Positive.
First published: 2024-02-01.

Intended use: Treatment of lymphatic malformations

Source: https://www.ema.europa.eu/en/medicines/human/orphan-designations/eu-3-23-2841